EMA orphan designation EU/3/26/3224
Active substance: surovatamig
Designated 2026-04-20. Orphan designation status: Positive.
First published: 2026-04-22.

Intended use: Treatment of large B-cell lymphoma

Source: https://www.ema.europa.eu/en/medicines/human/orphan-designations/eu-3-26-3224